EMA orphan designation EU/3/13/1153: Darzalex
Active substance: daratumumab
Designated 2013-07-17. Orphan designation status: Positive. EMA product number: EMEA/H/C/004077.
First published: 2013-08-16. Last updated: 2018-10-02.

Intended use: Treatment of plasma-cell myeloma

Source: https://www.ema.europa.eu/en/medicines/human/orphan-designations/eu-3-13-1153